EMA orphan designation EU/3/10/821: Heparesc
Active substance: human heterologous liver cells
Designated 2010-12-17. Orphan designation status: Positive. EMA product number: EMEA/H/C/003750.
First published: 2011-01-20. Last updated: 2016-08-16.

Intended use: Treatment of carbamoyl-phosphate synthase-1 deficiency

Source: https://www.ema.europa.eu/en/medicines/human/orphan-designations/eu-3-10-821